EMA orphan designation EU/3/06/381
Active substance: human monoclonal antibody against Pseudomonas aeruginosa serotype O11 (Panobacumab)
Designated 2006-06-29. Orphan designation status: Positive.
First published: 2009-05-15. Last updated: 2021-07-13.

Intended use: Treatment of pneumonia caused by serotype O11 Pseudomonas aeruginosa

Source: https://www.ema.europa.eu/en/medicines/human/orphan-designations/eu-3-06-381